EMA orphan designation EU/3/13/1218
Active substance: recombinant human type I pancreatic elastase for the prevention of arteriovenous access dysfunction in haemodialysis patients (vonapanitase)
Designated 2014-01-16. Orphan designation status: Withdrawn.
First published: 2014-01-23. Last updated: 2019-07-09.

Intended use: Prevention of arteriovenous access dysfunction in haemodialysis patients

Source: https://www.ema.europa.eu/en/medicines/human/orphan-designations/eu-3-13-1218